EMA orphan designation EU/3/18/2070
Active substance: (6aR,10aR)-3-(1,1-dimethylheptyl)-delta8-tetrahydro-cannabinol-9-carboxylic acid
Orphan designation status: Withdrawn.
First published: 2019-01-10. Last updated: 2024-04-09.

Intended use: Treatment of dermatomyositis

Source: https://www.ema.europa.eu/en/medicines/human/orphan-designations/eu-3-18-2070